EMA orphan designation EU/3/15/1558
Active substance: three chimeric human/murine monoclonal antibodies against the Ebola (Zaire) surface glycoprotein (porgaviximab)
Designated 2015-10-09. Orphan designation status: Withdrawn.
First published: 2015-11-18. Last updated: 2021-03-19.

Intended use: Treatment for Ebola virus disease

Source: https://www.ema.europa.eu/en/medicines/human/orphan-designations/eu-3-15-1558